EMA orphan designation EU/3/04/192
Designated 2004-03-08. Orphan designation status: Withdrawn.
First published: 2008-07-29. Last updated: 2020-01-13.

Intended use: Treatment of myelodysplastic syndromes

Source: https://www.ema.europa.eu/en/medicines/human/orphan-designations/eu-3-04-192